EMA orphan designation EU/3/25/3192
Active substance: opamtistomig
Designated 2026-01-09. Orphan designation status: Positive.
First published: 2026-04-10.

Intended use: Treatment of extrapulmonary neuroendocrine carcinoma

Source: https://www.ema.europa.eu/en/medicines/human/orphan-designations/eu-3-25-3192